EMA orphan designation EU/3/10/747
Active substance: N-{2-Chloro-4-[(6,7-dimethoxy-4-quinolyl)oxy]phenyl}-N'-(5-methyl-3-isoxazolyl) urea hydrochloride monohydrate
Designated 2010-06-09. Orphan designation status: Withdrawn.
First published: 2010-06-23. Last updated: 2017-05-17.

Intended use: Treatment of renal-cell carcinoma

Source: https://www.ema.europa.eu/en/medicines/human/orphan-designations/eu-3-10-747